EMA orphan designation EU/3/24/2943
Active substance: camrelizumab
Designated 2024-07-25. Orphan designation status: Positive.
First published: 2024-09-13. Last updated: 2024-10-22.

Intended use: Treatment of hepatocellular carcinoma

Source: https://www.ema.europa.eu/en/medicines/human/orphan-designations/eu-3-24-2943